EMA orphan designation EU/3/22/2651
Active substance: hydroquinidine hydrochloride
Designated 2022-07-18. Orphan designation status: Positive.
First published: 2023-01-23.

Intended use: Treatment of Brugada syndrome

Source: https://www.ema.europa.eu/en/medicines/human/orphan-designations/eu-3-22-2651